EMA orphan designation EU/3/13/1104
Active substance: terguride
Designated 2013-02-08. Orphan designation status: Withdrawn.
First published: 2013-03-04. Last updated: 2013-11-25.

Intended use: Treatment of systemic sclerosis

Source: https://www.ema.europa.eu/en/medicines/human/orphan-designations/eu-3-13-1104